EMA orphan designation EU/3/04/244: Infinia
Active substance: alpha-1 antitrypsin
Designated 2004-11-16. Orphan designation status: Positive. EMA product number: EMEA/H/C/003934.
First published: 2009-09-17. Last updated: 2019-05-07.

Intended use: Treatment of emphysema secondary to congenital alpha-1 antitrypsin deficiency

Source: https://www.ema.europa.eu/en/medicines/human/orphan-designations/eu-3-04-244